EMA orphan designation EU/3/14/1249: Waylivra
Active substance: phosphorothioate oligonucleotide targeted to apolipoprotein C-III (volanesorsen)
Designated 2014-02-19. Orphan designation status: Positive. EMA product number: EMEA/H/C/004538.
First published: 2014-04-03. Last updated: 2023-02-02.

Intended use: Treatment of familial chylomicronaemia syndrome

Source: https://www.ema.europa.eu/en/medicines/human/orphan-designations/eu-3-14-1249